EMA orphan designation EU/3/10/742
Active substance: 2-Methoxymethyl-2-hydroxymethyl-1-azabicyclo[2,2,2]octan-3-one
Designated 2010-06-10. Orphan designation status: Positive.
First published: 2010-06-25. Last updated: 2020-10-13.

Intended use: Treatment of acute myeloid leukaemia

Source: https://www.ema.europa.eu/en/medicines/human/orphan-designations/eu-3-10-742